EMA orphan designation EU/3/20/2382
Active substance: erlotinib
Designated 2020-12-09. Orphan designation status: Positive.
First published: 2021-05-27. Last updated: 2024-10-02.

Intended use: Treatment of patients with Olmsted syndrome

Source: https://www.ema.europa.eu/en/medicines/human/orphan-designations/eu-3-20-2382